EMA orphan designation EU/3/14/1277
Active substance: Plasmid DNA encoding the human cystic fibrosis transmembrane conductance regulator gene complexed with a non-viral, cationic lipid based gene transfer agent
Designated 2014-06-04. Orphan designation status: Positive.
First published: 2014-07-10. Last updated: 2014-07-10.

Intended use: Treatment of cystic fibrosis

Source: https://www.ema.europa.eu/en/medicines/human/orphan-designations/eu-3-14-1277